EMA orphan designation EU/3/24/2925
Active substance: efineptakin alfa
Designated 2024-05-24. Orphan designation status: Positive.
First published: 2024-08-22. Last updated: 2024-10-31.

Intended use: Treatment of acute radiation syndrome

Source: https://www.ema.europa.eu/en/medicines/human/orphan-designations/eu-3-24-2925